EMA orphan designation EU/3/12/1037
Active substance: Elotuzumab
Designated 2012-08-09. Orphan designation status: Withdrawn.
First published: 2012-09-26. Last updated: 2016-05-23.

Intended use: Treatment of multiple myeloma

Source: https://www.ema.europa.eu/en/medicines/human/orphan-designations/eu-3-12-1037